EMA orphan designation EU/3/03/152
Active substance: Engineered protein inhibitor of human neutrophil elastase
Designated 2003-07-09. Orphan designation status: Withdrawn.
First published: 2009-10-15. Last updated: 2009-10-15.

Intended use: Treatment of cystic fibrosis

Source: https://www.ema.europa.eu/en/medicines/human/orphan-designations/eu-3-03-152